EMA orphan designation EU/3/21/2516
Active substance: nadunolimab
Designated 2021-11-12. Orphan designation status: Positive.
First published: 2022-06-08.

Intended use: Treatment of pancreatic cancer

Source: https://www.ema.europa.eu/en/medicines/human/orphan-designations/eu-3-21-2516